EMA orphan designation EU/3/10/792
Active substance: Maytansinoid-conjugated humanised monoclonal antibody against CD56
Designated 2010-10-01. Orphan designation status: Withdrawn.
First published: 2010-10-14. Last updated: 2015-03-06.

Intended use: Treatment of small cell lung cancer

Source: https://www.ema.europa.eu/en/medicines/human/orphan-designations/eu-3-10-792